EMA orphan designation EU/3/14/1324
Active substance: 2-(2-Methyl-5-nitro-1H-imidazol-1-yl)ethylsulfamide
Designated 2014-08-22. Orphan designation status: Positive.
First published: 2014-10-02. Last updated: 2014-10-02.

Intended use: Treatment of small cell lung cancer

Source: https://www.ema.europa.eu/en/medicines/human/orphan-designations/eu-3-14-1324